EMA orphan designation EU/3/07/489
Active substance: ciclosporin
Designated 2007-10-29. Orphan designation status: Withdrawn.
First published: 2008-07-02. Last updated: 2015-05-13.

Intended use: Treatment of herpes simplex virus stromal keratitis

Source: https://www.ema.europa.eu/en/medicines/human/orphan-designations/eu-3-07-489